EMA orphan designation EU/3/10/807
Active substance: Methylthioninium
Designated 2010-11-26. Orphan designation status: Positive.
First published: 2010-12-15. Last updated: 2019-03-28.

Intended use: treatment of frontotemporal dementia with parkinsonism-17

Source: https://www.ema.europa.eu/en/medicines/human/orphan-designations/eu-3-10-807